EMA orphan designation EU/3/12/994
Active substance: yttrium (90Y)-DTPA-radiolabelled chimeric monoclonal antibody against frizzled homologue 10
Designated 2012-05-25. Orphan designation status: Positive.
First published: 2012-06-18. Last updated: 2012-06-18.

Intended use: Treatment of soft tissue sarcoma

Source: https://www.ema.europa.eu/en/medicines/human/orphan-designations/eu-3-12-994